EMA orphan designation EU/3/15/1482
Active substance: adeno-associated viral vector serotype 5 containing the human CHM gene
Designated 2015-04-24. Orphan designation status: Positive.
First published: 2015-05-22. Last updated: 2017-08-16.

Intended use: Treatment of choroideraemia

Source: https://www.ema.europa.eu/en/medicines/human/orphan-designations/eu-3-15-1482